EMA orphan designation EU/3/15/1585
Active substance: sirolimus
Designated 2015-12-14. Orphan designation status: Positive.
First published: 2016-02-02. Last updated: 2022-06-08.

Intended use: Treatment of beta thalassaemia intermedia and major

Source: https://www.ema.europa.eu/en/medicines/human/orphan-designations/eu-3-15-1585